EMA orphan designation EU/3/16/1690
Active substance: 16-base single-stranded peptide nucleic acid oligonucleotide linked to 7-amino acid peptide
Designated 2016-07-14. Orphan designation status: Positive.
First published: 2016-09-06. Last updated: 2016-09-06.

Intended use: Treatment of soft tissue sarcoma

Source: https://www.ema.europa.eu/en/medicines/human/orphan-designations/eu-3-16-1690